EMA orphan designation EU/3/17/1850
Active substance: Adeno-associated viral vector serotype LK03 encoding human ornithine transcarbamylase
Designated 2017-03-20. Orphan designation status: Positive.
First published: 2017-05-05. Last updated: 2023-03-27.

Intended use: Treatment of ornithine transcarbamylase deficiency

Source: https://www.ema.europa.eu/en/medicines/human/orphan-designations/eu-3-17-1850